EMA orphan designation EU/3/22/2636
Active substance: 2'-O-(2-methoxyethyl) modified antisense oligonucleotide targeting UBE3A antisense transcript RNA
Designated 2022-06-21. Orphan designation status: Positive.
First published: 2022-09-22.

Intended use: Treatment of Angelman syndrome

Source: https://www.ema.europa.eu/en/medicines/human/orphan-designations/eu-3-22-2636